EMA orphan designation EU/3/23/2749
Active substance: Fosmanogepix
Designated 2023-02-15. Orphan designation status: Positive.
First published: 2024-01-19. Last updated: 2024-10-02.

Intended use: Treatment of mucormycosis

Source: https://www.ema.europa.eu/en/medicines/human/orphan-designations/eu-3-23-2749